EMA orphan designation EU/3/13/1228
Active substance: obeticholic acid
Designated 2014-03-16. Orphan designation status: Withdrawn.
First published: 2014-03-24. Last updated: 2022-05-10.

Intended use: Treatment of primary sclerosing cholangitis

Source: https://www.ema.europa.eu/en/medicines/human/orphan-designations/eu-3-13-1228